EMA orphan designation EU/3/14/1319
Active substance: recombinant factor VIIa modified with three terminal repeats derived from the β chain of human chorionic gonadotropin
Designated 2014-08-22. Orphan designation status: Positive.
First published: 2014-10-01. Last updated: 2021-09-16.

Intended use: Treatment of haemophilia B

Source: https://www.ema.europa.eu/en/medicines/human/orphan-designations/eu-3-14-1319